EMA orphan designation EU/3/12/985
Active substance: recombinant human methionine proinsulin
Designated 2012-04-26. Orphan designation status: Positive.
First published: 2012-06-18. Last updated: 2012-06-18.

Intended use: Treatment of retinitis pigmentosa

Source: https://www.ema.europa.eu/en/medicines/human/orphan-designations/eu-3-12-985